EMA orphan designation EU/3/22/2704
Active substance: sodium ({(2S)-1,4-bis[2-(4-chloro-3-fluorophenoxy)acetamido]bicyclo[2.2.2]octan-2-yl}oxy)methyl hydrogen phosphate-2-amino-2-(hydroxymethyl)propane-1,3-diol (1/1/1)
Designated 2022-10-11. Orphan designation status: Positive.
First published: 2023-01-24.

Intended use: Treatment of vanishing white matter disease

Source: https://www.ema.europa.eu/en/medicines/human/orphan-designations/eu-3-22-2704